EMA orphan designation EU/3/10/770
Active substance: 1-[2-(Benzo[1,2,5]thiadiazol-5-ylamino)-6-(2,6-dichloro-phenyl)-pyrido[2,3-d]pyrimidin-7-yl]-3-tert-butyl-urea
Designated 2010-09-20. Orphan designation status: Withdrawn.
First published: 2010-10-18. Last updated: 2015-03-06.

Intended use: Treatment of acute myeloid leukaemia

Source: https://www.ema.europa.eu/en/medicines/human/orphan-designations/eu-3-10-770